EMA orphan designation EU/3/25/3188
Active substance: Phe-Thr-Thr-Phe-Thr-Val-Thr
Designated 2026-01-09. Orphan designation status: Positive.
First published: 2026-04-10.

Intended use: Treatment of idiopathic pulmonary fibrosis

Source: https://www.ema.europa.eu/en/medicines/human/orphan-designations/eu-3-25-3188